EMA orphan designation EU/3/12/1055
Active substance: belinostat
Designated 2012-10-10. Orphan designation status: Positive.
First published: 2012-11-16. Last updated: 2016-02-08.

Intended use: Treatment of peripheral T-cell lymphoma (nodal, other extranodal and leukaemic/disseminated)

Source: https://www.ema.europa.eu/en/medicines/human/orphan-designations/eu-3-12-1055